EMA orphan designation EU/3/05/317
Active substance: anti-CD3 mAb (SPV-T3a)-ricin A chain fusion protein; anti-CD7 mAb (WT1)-ricin A chain fusion protein
Designated 2005-08-26. Orphan designation status: Positive.
First published: 2005-10-27. Last updated: 2024-04-11.

Intended use: Treatment of graft-versus-host disease

Source: https://www.ema.europa.eu/en/medicines/human/orphan-designations/eu-3-05-317